EMA orphan designation EU/3/04/225
Active substance: sodium dichloroacetate
Designated 2004-09-02. Orphan designation status: Withdrawn.
First published: 2009-10-15. Last updated: 2009-10-15.

Intended use: Treatment of systemic monochloroacetate poisoning

Source: https://www.ema.europa.eu/en/medicines/human/orphan-designations/eu-3-04-225